EMA orphan designation EU/3/17/1969
Active substance: recombinant adeno-associated viral vector serotype 2/1 encoding human beta-hexosaminidase alpha and beta subunits
Designated 2018-01-17. Orphan designation status: Positive.
First published: 2018-03-13. Last updated: 2018-03-13.

Intended use: Treatment of GM2 gangliosidosis

Source: https://www.ema.europa.eu/en/medicines/human/orphan-designations/eu-3-17-1969